EMA orphan designation EU/3/11/890
Active substance: peretinoin
Designated 2011-08-05. Orphan designation status: Positive.
First published: 2011-08-16. Last updated: 2018-07-26.

Intended use: Treatment of hepatocellular carcinoma

Source: https://www.ema.europa.eu/en/medicines/human/orphan-designations/eu-3-11-890